EMA orphan designation EU/3/23/2835: Kinselby
Active substance: resminostat
Orphan designation status: Positive. EMA product number: EMEA/H/C/006259.
First published: 2024-01-24.

Intended use: Treatment of cutaneous T-cell lymphoma

Source: https://www.ema.europa.eu/en/medicines/human/orphan-designations/eu-3-23-2835